EMA orphan designation EU/3/21/2426
Active substance: ganglioside GM1
Designated 2021-04-13. Orphan designation status: Positive.
First published: 2022-01-24. Last updated: 2024-10-23.

Intended use: Treatment of amyotrophic lateral sclerosis

Source: https://www.ema.europa.eu/en/medicines/human/orphan-designations/eu-3-21-2426